EMA orphan designation EU/3/05/322
Active substance: alfimeprase
Designated 2005-10-28. Orphan designation status: Withdrawn.
First published: 2008-07-29. Last updated: 2008-07-29.

Intended use: Treatment of acute peripheral arterial occlusion

Source: https://www.ema.europa.eu/en/medicines/human/orphan-designations/eu-3-05-322